EMA orphan designation EU/3/18/1984
Active substance: recombinant human monoclonal antibody against mannan-binding lectin-associated serine protease-2
Designated 2018-02-22. Orphan designation status: Positive.
First published: 2018-04-11. Last updated: 2018-04-11.

Intended use: Treatment of primary IgA nephropathy

Source: https://www.ema.europa.eu/en/medicines/human/orphan-designations/eu-3-18-1984